EMA orphan designation EU/3/13/1173
Active substance: Idelalisib
Designated 2013-08-05. Orphan designation status: Withdrawn.
First published: 2013-09-13. Last updated: 2013-11-18.

Intended use: Treatment of chronic lymphocytic leukaemia / small lymphocytic lymphoma

Source: https://www.ema.europa.eu/en/medicines/human/orphan-designations/eu-3-13-1173